EMA orphan designation EU/3/12/1092
Active substance: chimeric monoclonal antibody against claudin 6
Designated 2013-01-24. Orphan designation status: Withdrawn.
First published: 2013-03-01. Last updated: 2021-04-08.

Intended use: Treatment of ovarian cancer

Source: https://www.ema.europa.eu/en/medicines/human/orphan-designations/eu-3-12-1092